EMA orphan designation EU/3/15/1527
Active substance: Hydrocinnamate-[Orn-Pro-dCha-Trp-Arg]acetate
Designated 2015-07-28. Orphan designation status: Positive.
First published: 2015-08-18. Last updated: 2015-08-18.

Intended use: Treatment of amyotrophic lateral sclerosis

Source: https://www.ema.europa.eu/en/medicines/human/orphan-designations/eu-3-15-1527